EMA orphan designation EU/3/12/1042
Active substance: Covalently closed DNA plasmids coding for cytomegalovirus phosphoprotein 65 and glycoprotein B genes
Designated 2012-08-09. Orphan designation status: Withdrawn.
First published: 2012-09-26. Last updated: 2019-02-21.

Intended use: Prevention of cytomegalovirus disease in patients with impaired cell-mediated immunity

Source: https://www.ema.europa.eu/en/medicines/human/orphan-designations/eu-3-12-1042